EMA orphan designation EU/3/14/1275
Active substance: aganirsen
Designated 2014-06-10. Orphan designation status: Positive.
First published: 2014-07-10. Last updated: 2014-07-10.

Intended use: Treatment of central retinal vein occlusion

Source: https://www.ema.europa.eu/en/medicines/human/orphan-designations/eu-3-14-1275